EMA orphan designation EU/3/19/2162
Active substance: emixustat hydrochloride
Designated 2019-05-29. Orphan designation status: Positive.
First published: 2019-08-09.

Intended use: Treatment of Stargardt's disease

Source: https://www.ema.europa.eu/en/medicines/human/orphan-designations/eu-3-19-2162